EMA orphan designation EU/3/15/1513
Active substance: Doxorubicin
Designated 2015-07-28. Orphan designation status: Positive.
First published: 2015-08-18. Last updated: 2015-08-18.

Intended use: Treatment of hepatoblastoma

Source: https://www.ema.europa.eu/en/medicines/human/orphan-designations/eu-3-15-1513